EMA orphan designation EU/3/05/343
Active substance: enzastaurin hydrochloride
Orphan designation status: Withdrawn.
First published: 2006-08-08. Last updated: 2024-10-16.

Intended use: Treatment of glioma

Source: https://www.ema.europa.eu/en/medicines/human/orphan-designations/eu-3-05-343